EMA orphan designation EU/3/12/1094: Voxzogo
Active substance: modified recombinant human C-type natriuretic peptide (Vosoritide)
Designated 2013-01-24. Orphan designation status: Positive. EMA product number: EMEA/H/C/005475.
First published: 2013-03-01. Last updated: 2021-09-09.

Intended use: Treatment of achondroplasia

Source: https://www.ema.europa.eu/en/medicines/human/orphan-designations/eu-3-12-1094